EMA orphan designation EU/3/25/3165
Active substance: autologous peripheral blood-derived CD34+ haematopoietic stem and progenitor cells transduced with a lentiviral vector containing the human MAN2B1 gene
Designated 2025-09-12. Orphan designation status: Positive.
First published: 2026-03-26.

Intended use: Treatment of alpha-mannosidosis

Source: https://www.ema.europa.eu/en/medicines/human/orphan-designations/eu-3-25-3165